EMA orphan designation EU/3/25/3145
Active substance: soquelitinib
Designated 2025-10-20. Orphan designation status: Positive.
First published: 2026-04-14.

Intended use: Treatment of peripheral T-cell lymphoma

Source: https://www.ema.europa.eu/en/medicines/human/orphan-designations/eu-3-25-3145